EMA orphan designation EU/3/11/898: Xegafri
Active substance: sirolimus
Designated 2011-08-30. Orphan designation status: Withdrawn. EMA product number: EMEA/H/C/004053.
First published: 2011-09-21. Last updated: 2022-08-30.

Intended use: Treatment of chronic non-infectious uveitis

Source: https://www.ema.europa.eu/en/medicines/human/orphan-designations/eu-3-11-898